EMA orphan designation EU/3/17/1932
Active substance: synthetic cyclic 8 amino acid analogue of human unacylated ghrelin
Designated 2017-10-16. Orphan designation status: Positive.
First published: 2018-01-08. Last updated: 2018-01-08.

Intended use: Treatment of Prader-Willi syndrome

Source: https://www.ema.europa.eu/en/medicines/human/orphan-designations/eu-3-17-1932